EMA orphan designation EU/3/24/2907
Active substance: sevasemten
Designated 2024-03-21. Orphan designation status: Positive.
First published: 2024-07-23.

Intended use: Treatment of Becker muscular dystrophy

Source: https://www.ema.europa.eu/en/medicines/human/orphan-designations/eu-3-24-2907